EMA orphan designation EU/3/23/2827
Active substance: mitapivat sulfate
Designated 2023-10-13. Orphan designation status: Positive.
First published: 2024-02-01.

Intended use: Treatment of thalassaemia intermedia and major

Source: https://www.ema.europa.eu/en/medicines/human/orphan-designations/eu-3-23-2827